EMA orphan designation EU/3/06/368: Galafold
Active substance: 1-Deoxygalactonojirimycin hydrochloride
Designated 2006-05-22. Orphan designation status: Positive. EMA product number: EMEA/H/C/004059.
First published: 2009-05-15. Last updated: 2019-05-21.

Intended use: Treatment of Fabry disease

Source: https://www.ema.europa.eu/en/medicines/human/orphan-designations/eu-3-06-368